EMA orphan designation EU/3/18/2124
Active substance: acetylleucine
Designated 2019-01-11. Orphan designation status: Positive.
First published: 2019-03-27. Last updated: 2019-04-25.

Intended use: Treatment of ataxia telangiectasia

Source: https://www.ema.europa.eu/en/medicines/human/orphan-designations/eu-3-18-2124